EMA orphan designation EU/3/25/3190
Active substance: domatinostat
Designated 2026-01-09. Orphan designation status: Positive.
First published: 2026-04-10.

Intended use: Treatment of osteosarcoma

Source: https://www.ema.europa.eu/en/medicines/human/orphan-designations/eu-3-25-3190